EMA orphan designation EU/3/07/445
Active substance: antisense oligonucleotide (TATCCGGAGGGCTCGCCATGCTGCT)
Designated 2007-04-17. Orphan designation status: Positive.
First published: 2007-12-04. Last updated: 2007-12-04.

Intended use: Prevention of corneal graft rejection

Source: https://www.ema.europa.eu/en/medicines/human/orphan-designations/eu-3-07-445